EMA orphan designation EU/3/16/1716
Active substance: adeno-associated viral vector serotype 9 containing the human mini-dystrophin gene
Designated 2016-08-29. Orphan designation status: Withdrawn.
First published: 2016-10-03. Last updated: 2026-05-18.

Intended use: Treatment of Duchenne muscular dystrophy

Source: https://www.ema.europa.eu/en/medicines/human/orphan-designations/eu-3-16-1716